EMA orphan designation EU/3/25/3140
Active substance: risvutatug rezetecan
Designated 2025-10-20. Orphan designation status: Positive.
First published: 2026-04-14.

Intended use: Treatment of pulmonary neuroendocrine carcinoma

Source: https://www.ema.europa.eu/en/medicines/human/orphan-designations/eu-3-25-3140